EMA orphan designation EU/3/22/2743: Zynyz
Active substance: retifanlimab
Designated 2023-01-13. Orphan designation status: Positive. EMA product number: EMEA/H/C/006194.
First published: 2023-03-23.

Intended use: Treatment of Merkel cell carcinoma

Source: https://www.ema.europa.eu/en/medicines/human/orphan-designations/eu-3-22-2743